EMA orphan designation EU/3/13/1234
Active substance: N-(3-(5-fluoro-2-(4-(2-methoxyethoxy)phenylamino)pyrimidin-4-ylamino)phenyl)acrylamide benzenesulfonic acid salt
Designated 2014-03-16. Orphan designation status: Withdrawn.
First published: 2014-03-24. Last updated: 2015-03-06.

Intended use: Treatment of chronic lymphocytic leukaemia / small lymphocytic lymphoma

Source: https://www.ema.europa.eu/en/medicines/human/orphan-designations/eu-3-13-1234